EMA orphan designation EU/3/17/1899
Active substance: antisense oligonucleotide targeting exon 13 in the USH2A gene
Designated 2017-08-23. Orphan designation status: Positive.
First published: 2017-10-17. Last updated: 2017-10-17.

Intended use: Treatment of retinitis pigmentosa

Source: https://www.ema.europa.eu/en/medicines/human/orphan-designations/eu-3-17-1899